EMA orphan designation EU/3/16/1730: Prohippur
Active substance: sodium benzoate
Designated 2016-08-29. Orphan designation status: Positive. EMA product number: EMEA/H/C/004150.
First published: 2016-10-03. Last updated: 2016-10-03.

Intended use: Treatment of ornithine translocase deficiency

Source: https://www.ema.europa.eu/en/medicines/human/orphan-designations/eu-3-16-1730